EMA orphan designation EU/3/22/2668
Active substance: erlotinib
Designated 2022-07-18. Orphan designation status: Positive.
First published: 2023-01-23. Last updated: 2024-10-02.

Intended use: Treatment of pachyonychia congenita

Source: https://www.ema.europa.eu/en/medicines/human/orphan-designations/eu-3-22-2668